EMA orphan designation EU/3/23/2870
Active substance: selumetinib
Designated 2023-12-13. Orphan designation status: Positive.
First published: 2024-02-08.

Intended use: Treatment of amyotrophic lateral sclerosis

Source: https://www.ema.europa.eu/en/medicines/human/orphan-designations/eu-3-23-2870